EMA orphan designation EU/3/21/2513
Active substance: psilocybine
Designated 2021-10-15. Orphan designation status: Positive.
First published: 2022-01-21.

Intended use: Treatment of fragile X syndrome

Source: https://www.ema.europa.eu/en/medicines/human/orphan-designations/eu-3-21-2513